EMA orphan designation EU/3/16/1783
Active substance: N-(5-(6-chloro-2,2-difluorobenzo[d][1,3]dioxol-5-yl)pyrazin-2-yl)-2-fluoro-6-methylbenzamide
Designated 2016-11-18. Orphan designation status: Positive.
First published: 2016-12-13. Last updated: 2018-07-20.

Intended use: Treatment of acute pancreatitis

Source: https://www.ema.europa.eu/en/medicines/human/orphan-designations/eu-3-16-1783